EMA orphan designation EU/3/23/2861
Active substance: (S)-N-(1-(3-Fluoro-2'-methoxy-[1,1'-biphenyl]-4-yl)-2-oxopiperidin-3-yl)-5-(pyridin-2-yl)thiophene-2-sulfonamide
Designated 2023-12-13. Orphan designation status: Positive.
First published: 2024-02-01. Last updated: 2024-10-23.

Intended use: Treatment of soft-tissue sarcoma

Source: https://www.ema.europa.eu/en/medicines/human/orphan-designations/eu-3-23-2861